EMA orphan designation EU/3/23/2797
Active substance: vactosertib
Designated 2023-07-25. Orphan designation status: Positive.
First published: 2024-02-01.

Intended use: Treatment of osteosarcoma

Source: https://www.ema.europa.eu/en/medicines/human/orphan-designations/eu-3-23-2797